EMA orphan designation EU/3/24/3022
Active substance: allogenic umbilical cord-derived osteoblast cells
Designated 2025-01-16. Orphan designation status: Positive.
First published: 2025-02-20.

Intended use: Treatment of non-traumatic osteonecrosis

Source: https://www.ema.europa.eu/en/medicines/human/orphan-designations/eu-3-24-3022